EMA orphan designation EU/3/11/857: Onpattro
Active substance: synthetic double-stranded siRNA oligonucleotide directed against transthyretin mRNA (also known as patisiran)
Orphan designation status: Positive. EMA product number: EMEA/H/C/004699.
First published: 2011-04-26. Last updated: 2018-05-30.

Intended use: Treatment of transthyretin-mediated amyloidosis

Source: https://www.ema.europa.eu/en/medicines/human/orphan-designations/eu-3-11-857